EMA orphan designation EU/3/20/2395
Active substance: celecoxib; ciprofloxacin
Designated 2021-01-06. Orphan designation status: Positive.
First published: 2021-05-18. Last updated: 2023-09-19.

Intended use: Treatment of amyotrophic lateral sclerosis

Source: https://www.ema.europa.eu/en/medicines/human/orphan-designations/eu-3-20-2395